EMA orphan designation EU/3/13/1117
Active substance: ramiprilat
Designated 2013-03-12. Orphan designation status: Withdrawn.
First published: 2013-04-05. Last updated: 2025-06-30.

Intended use: Treatment of Stargardt's disease

Source: https://www.ema.europa.eu/en/medicines/human/orphan-designations/eu-3-13-1117